EMA orphan designation EU/3/22/2732
Active substance: autologous T-cells transduced with a lentiviral vector encoding a chimeric antigen receptor against CD7
Designated 2022-12-09. Orphan designation status: Positive.
First published: 2023-03-13. Last updated: 2023-03-14.

Intended use: Treatment of acute lymphoblastic leukaemia

Source: https://www.ema.europa.eu/en/medicines/human/orphan-designations/eu-3-22-2732